EMA orphan designation EU/3/16/1798
Active substance: avelumab
Designated 2016-12-12. Orphan designation status: Withdrawn.
First published: 2017-01-09. Last updated: 2019-10-15.

Intended use: Treatment of gastric cancer

Source: https://www.ema.europa.eu/en/medicines/human/orphan-designations/eu-3-16-1798